EMA orphan designation EU/3/19/2243
Active substance: motixafortide
Designated 2020-01-09. Orphan designation status: Positive.
First published: 2020-05-19.

Intended use: Treatment of pancreatic cancer

Source: https://www.ema.europa.eu/en/medicines/human/orphan-designations/eu-3-19-2243